EMA orphan designation EU/3/21/2508
Active substance: allogeneic umbilical cord mesenchymal cells-derived extracellular vesicles
Designated 2021-10-15. Orphan designation status: Positive.
First published: 2022-01-21.

Intended use: Prevention of bronchopulmonary dysplasia

Source: https://www.ema.europa.eu/en/medicines/human/orphan-designations/eu-3-21-2508